EMA orphan designation EU/3/16/1741
Active substance: adeno-associated viral vector serotype 5 containing the human RLBP1 gene
Designated 2016-10-14. Orphan designation status: Positive.
First published: 2016-11-15. Last updated: 2022-08-09.

Intended use: Treatment of retinitis pigmentosa

Source: https://www.ema.europa.eu/en/medicines/human/orphan-designations/eu-3-16-1741